EMA orphan designation EU/3/11/896
Active substance: multilamellar microvesicle comprising phosphatidylcholine, sphingomyelin, phosphatidylethanolamine, phosphatidylserine, phosphatidylinositol and cholesterol
Designated 2011-08-30. Orphan designation status: Withdrawn.
First published: 2011-09-21. Last updated: 2022-04-06.

Intended use: Treatment of cystic fibrosis

Source: https://www.ema.europa.eu/en/medicines/human/orphan-designations/eu-3-11-896